EMA orphan designation EU/3/21/2405
Active substance: autologous T lymphocyte-enriched population of cells transduced with a lentiviral vector encoding a chimeric antigen receptor targeting human claudin 18.2 antigen with CD28 and CD3-zeta intracellular signalling domains
Designated 2021-02-19. Orphan designation status: Positive.
First published: 2022-02-23. Last updated: 2025-06-05.

Intended use: Treatment of gastric cancer

Source: https://www.ema.europa.eu/en/medicines/human/orphan-designations/eu-3-21-2405